EMA orphan designation EU/3/03/184
Active substance: cilengitide
Designated 2004-01-15. Orphan designation status: Withdrawn.
First published: 2004-07-08. Last updated: 2013-04-09.

Intended use: Treatment of glioma

Source: https://www.ema.europa.eu/en/medicines/human/orphan-designations/eu-3-03-184